EMA orphan designation EU/3/25/3043
Active substance: povetacicept
Designated 2025-03-25. Orphan designation status: Positive.
First published: 2025-04-07.

Intended use: Treatment of primary IgA nephropathy

Source: https://www.ema.europa.eu/en/medicines/human/orphan-designations/eu-3-25-3043